EMA orphan designation EU/3/25/3062
Active substance: adeno-associated viral vector serotype 2i8 containing the human LAMP2 isoform B transgene
Designated 2025-05-22. Orphan designation status: Positive.
First published: 2025-06-24.

Intended use: Treatment of Danon disease

Source: https://www.ema.europa.eu/en/medicines/human/orphan-designations/eu-3-25-3062